EMA orphan designation EU/3/20/2290
Active substance: stiripentol
Designated 2020-06-26. Orphan designation status: Positive.
First published: 2020-09-22.

Intended use: Treatment of primary hyperoxaluria

Source: https://www.ema.europa.eu/en/medicines/human/orphan-designations/eu-3-20-2290